EMA orphan designation EU/3/04/247
Active substance: 17-allylamino-17-demethoxygeldanamycin
Designated 2004-12-21. Orphan designation status: Withdrawn.
First published: 2009-09-17. Last updated: 2009-09-17.

Intended use: Treatment of multiple myeloma

Source: https://www.ema.europa.eu/en/medicines/human/orphan-designations/eu-3-04-247